EMA orphan designation EU/3/16/1764
Active substance: tetrofosmin
Designated 2016-09-20. Orphan designation status: Positive.
First published: 2016-11-15. Last updated: 2016-11-15.

Intended use: Diagnosis of glioma

Source: https://www.ema.europa.eu/en/medicines/human/orphan-designations/eu-3-16-1764